EMA orphan designation EU/3/09/636
Active substance: Dexamethasone phosphate
Designated 2009-05-15. Orphan designation status: Withdrawn.
First published: 2009-06-09. Last updated: 2016-10-21.

Intended use: Treatment of corneal graft rejection

Source: https://www.ema.europa.eu/en/medicines/human/orphan-designations/eu-3-09-636